EMA orphan designation EU/3/04/229
Active substance: Doxorubicine polyisohexylcyanoacrylate nanoparticles
Designated 2004-10-21. Orphan designation status: Withdrawn.
First published: 2009-09-21. Last updated: 2019-07-09.

Intended use: Treatment of hepatocellular carcinoma

Source: https://www.ema.europa.eu/en/medicines/human/orphan-designations/eu-3-04-229